EMA orphan designation EU/3/15/1438
Active substance: allogeneic CD4+ and CD8+ T lymphocytes ex vivo incubated with synthetic peptides of the viral antigens of cytomegalovirus, adenovirus and Epstein-Barr virus
Designated 2015-03-19. Orphan designation status: Positive.
First published: 2015-05-19. Last updated: 2015-05-19.

Intended use: Treatment of adenovirus infection following haematopoietic stem cell transplantation

Source: https://www.ema.europa.eu/en/medicines/human/orphan-designations/eu-3-15-1438